EMA orphan designation EU/3/01/045
Active substance: Betaine anhydrous
Designated 2001-07-09. Orphan designation status: Withdrawn.
First published: 2009-05-15. Last updated: 2017-07-17.

Intended use: Treatment of homocystinuria

Source: https://www.ema.europa.eu/en/medicines/human/orphan-designations/eu-3-01-045